EMA orphan designation EU/3/09/689
Active substance: Peptides mimicking antigen receptors on autoimmune B cells and autoimmune T cells associated with myasthenia gravis
Designated 2009-11-09. Orphan designation status: Positive.
First published: 2009-11-18. Last updated: 2014-10-07.

Intended use: Treatment of myasthenia gravis

Source: https://www.ema.europa.eu/en/medicines/human/orphan-designations/eu-3-09-689